EMA orphan designation EU/3/21/2422
Active substance: Lorcaserin hydrochloride
Designated 2021-03-26. Orphan designation status: Positive.
First published: 2022-01-19.

Intended use: Treatment of Dravet syndrome

Source: https://www.ema.europa.eu/en/medicines/human/orphan-designations/eu-3-21-2422